EMA orphan designation EU/3/17/1882
Active substance: Ibutamoren mesilate
Designated 2017-06-20. Orphan designation status: Positive.
First published: 2017-07-17. Last updated: 2021-09-02.

Intended use: Treatment of growth hormone deficiency

Source: https://www.ema.europa.eu/en/medicines/human/orphan-designations/eu-3-17-1882